EMA orphan designation EU/3/13/1105
Active substance: Humanised monoclonal antibody against myostatin (domagrozumab)
Designated 2013-02-08. Orphan designation status: Withdrawn.
First published: 2013-03-04. Last updated: 2020-10-23.

Intended use: Treatment of Duchenne muscular dystrophy

Source: https://www.ema.europa.eu/en/medicines/human/orphan-designations/eu-3-13-1105